EMA orphan designation EU/3/23/2842
Active substance: Glycerol phenylbutyrate
Designated 2023-10-13. Orphan designation status: Positive.
First published: 2024-01-24.

Intended use: Treatment of STXBP1 developmental and epileptic encephalopathy

Source: https://www.ema.europa.eu/en/medicines/human/orphan-designations/eu-3-23-2842